EMA orphan designation EU/3/10/779
Active substance: eflornithine
Designated 2010-09-20. Orphan designation status: Positive.
First published: 2010-10-18. Last updated: 2019-04-29.

Intended use: Treatment of familial adenomatous polyposis (FAP)

Source: https://www.ema.europa.eu/en/medicines/human/orphan-designations/eu-3-10-779